EMA orphan designation EU/3/25/3042
Active substance: extract from Cannabis flower, containing high levels of cannabidiolic acid and
Designated 2025-03-25. Orphan designation status: Positive.
First published: 2025-04-07.

Intended use: Treatment of Rett syndrome

Source: https://www.ema.europa.eu/en/medicines/human/orphan-designations/eu-3-25-3042